EMA orphan designation EU/3/14/1386
Active substance: 2-hydroxymethyl-2-methoxymethyl-1-azabicyclo[2,2,2]octan-3-one
Designated 2014-12-16. Orphan designation status: Positive.
First published: 2015-02-26. Last updated: 2020-10-13.

Intended use: Treatment of ovarian cancer

Source: https://www.ema.europa.eu/en/medicines/human/orphan-designations/eu-3-14-1386